EMA orphan designation EU/3/20/2273
Active substance: anti-(integrin beta-3) human monoclonal antibody
Designated 2020-04-22. Orphan designation status: Positive.
First published: 2020-08-13.

Intended use: Prevention of fetal and neonatal alloimmune thrombocytopenia due to human platelet antigen-1a incompatibility

Source: https://www.ema.europa.eu/en/medicines/human/orphan-designations/eu-3-20-2273